EMA orphan designation EU/3/15/1552
Active substance: autologous T cells transduced with retroviral vector encoding an anti-CD19 CD28/CD3-zeta chimeric antigen receptor (axicabtagene ciloleucel)
Designated 2015-10-09. Orphan designation status: Withdrawn.
First published: 2015-11-16. Last updated: 2022-01-12.

Intended use: Treatment of mantle cell lymphoma

Source: https://www.ema.europa.eu/en/medicines/human/orphan-designations/eu-3-15-1552